EMA orphan designation EU/3/22/2688
Active substance: tamibarotene
Designated 2022-08-10. Orphan designation status: Positive.
First published: 2022-12-15.

Intended use: Treatment of myelodysplastic syndromes

Source: https://www.ema.europa.eu/en/medicines/human/orphan-designations/eu-3-22-2688